EMA orphan designation EU/3/01/051: Kiacta
Active substance: 1,3-Propanedisulfonic acid, disodium salt
Designated 2001-07-31. Orphan designation status: Positive. EMA product number: EMEA/H/C/000779.
First published: 2009-05-15. Last updated: 2013-09-19.

Intended use: Treatment of systemic secondary amyloidosis

Source: https://www.ema.europa.eu/en/medicines/human/orphan-designations/eu-3-01-051